EMA orphan designation EU/3/04/245: Aplidin
Active substance: aplidine
Designated 2004-11-16. Orphan designation status: Positive. EMA product number: EMEA/H/C/004354.
First published: 2005-10-11. Last updated: 2005-10-11.

Intended use: Treatment of multiple myeloma

Source: https://www.ema.europa.eu/en/medicines/human/orphan-designations/eu-3-04-245